EMA orphan designation EU/3/13/1125
Active substance: (S)-3-(1-(9H-purin-6-ylamino)ethyl)-8-chloro-2-phenylisoquinolin-1(2H)-one (duvelisib)
Designated 2013-04-26. Orphan designation status: Positive.
First published: 2013-05-14. Last updated: 2021-05-31.

Intended use: Treatment of chronic lymphocytic leukaemia / small lymphocytic lymphoma

Source: https://www.ema.europa.eu/en/medicines/human/orphan-designations/eu-3-13-1125